EMA orphan designation EU/3/15/1533
Active substance: human allogeneic bone-marrow-derived osteoblastic cells
Designated 2015-08-10. Orphan designation status: Positive.
First published: 2015-10-02. Last updated: 2022-12-22.

Intended use: Treatment of osteogenesis imperfecta

Source: https://www.ema.europa.eu/en/medicines/human/orphan-designations/eu-3-15-1533